EMA orphan designation EU/3/19/2163
Active substance: N-(trans-3-(5-((R)-1-hydroxyethyl)-1,3,4-oxadiazol-2-yl)cyclobutyl)-3-phenylisoxazole-5-carboxamide
Designated 2019-05-29. Orphan designation status: Withdrawn.
First published: 2019-08-09. Last updated: 2021-07-30.

Intended use: Treatment of cystic fibrosis

Source: https://www.ema.europa.eu/en/medicines/human/orphan-designations/eu-3-19-2163